EMA orphan designation EU/3/14/1240
Active substance: cysteamine
Designated 2014-02-19. Orphan designation status: Positive.
First published: 2014-04-01. Last updated: 2017-04-20.

Intended use: Treatment of cystic fibrosis

Source: https://www.ema.europa.eu/en/medicines/human/orphan-designations/eu-3-14-1240